EMA orphan designation EU/3/18/2044
Active substance: allogeneic bone marrow derived mesenchymal stromal cells, ex-vivo expanded
Designated 2018-07-31. Orphan designation status: Positive.
First published: 2018-10-10.

Intended use: Treatment of graft-versus-host disease

Source: https://www.ema.europa.eu/en/medicines/human/orphan-designations/eu-3-18-2044